EMA orphan designation EU/3/04/257
Active substance: recombinant human bile-salt-stimulated lipase
Designated 2005-01-26. Orphan designation status: Withdrawn.
First published: 2005-10-11. Last updated: 2016-08-10.

Intended use: Treatment of cystic fibrosis

Source: https://www.ema.europa.eu/en/medicines/human/orphan-designations/eu-3-04-257